EMA orphan designation EU/3/02/116
Active substance: autologous Renal Cell Tumour Vaccine
Designated 2002-10-21. Orphan designation status: Positive.
First published: 2003-01-08. Last updated: 2003-01-08.

Intended use: Treatment of renal-cell carcinoma

Source: https://www.ema.europa.eu/en/medicines/human/orphan-designations/eu-3-02-116